EMA orphan designation EU/3/20/2331
Active substance: teclistamab
Orphan designation status: Withdrawn.
First published: 2021-02-11. Last updated: 2022-10-13.

Intended use: Treatment of multiple myeloma

Source: https://www.ema.europa.eu/en/medicines/human/orphan-designations/eu-3-20-2331